EMA orphan designation EU/3/17/1916
Active substance: 5-amino-1-(2-methyl-1H-benzo[d]imidazol-5-yl)-1H-pyrazol-4-yl 1H-indol-2-yl ketone mono[(S)-2-hydroxysuccinate]
Designated 2017-10-16. Orphan designation status: Withdrawn.
First published: 2018-01-10. Last updated: 2020-12-11.

Intended use: Treatment of biliary tract cancer

Source: https://www.ema.europa.eu/en/medicines/human/orphan-designations/eu-3-17-1916